EMA orphan designation EU/3/08/603
Active substance: pralatrexate
Designated 2009-01-19. Orphan designation status: Positive.
First published: 2009-06-29. Last updated: 2011-04-06.

Intended use: Treatment of non-papillary transitional cell carcinoma of the urinary bladder

Source: https://www.ema.europa.eu/en/medicines/human/orphan-designations/eu-3-08-603